EMA orphan designation EU/3/20/2277
Active substance: autologous T lymphocyte-enriched population of cells transduced with a lentiviral vector encoding a chimeric antigen receptor targeting human B cell maturation antigen with 4-1BB and CD3-zeta intracellular signalling domains
Designated 2020-06-04. Orphan designation status: Withdrawn.
First published: 2020-08-19. Last updated: 2025-04-04.

Intended use: Treatment of multiple myeloma

Source: https://www.ema.europa.eu/en/medicines/human/orphan-designations/eu-3-20-2277